EMA orphan designation EU/3/18/2056
Active substance: (S)-(−)-3-(4-aminophenyl)-2-methoxypropanoic acid
Designated 2018-08-24. Orphan designation status: Withdrawn.
First published: 2018-12-19.

Intended use: Treatment of idiopathic pulmonary fibrosis

Source: https://www.ema.europa.eu/en/medicines/human/orphan-designations/eu-3-18-2056